EMA orphan designation EU/3/11/931
Active substance: Plerixafor
Designated 2011-12-09. Orphan designation status: Withdrawn.
First published: 2011-12-20. Last updated: 2015-03-06.

Intended use: Adjunctive treatment to cytotoxic therapy in acute myeloid leukaemia

Source: https://www.ema.europa.eu/en/medicines/human/orphan-designations/eu-3-11-931